EMA orphan designation EU/3/26/3210
Active substance: tacrolimus monohydrate
Designated 2026-03-25. Orphan designation status: Positive.
First published: 2026-04-22.

Intended use: Treatment of vernal keratoconjunctivitis

Source: https://www.ema.europa.eu/en/medicines/human/orphan-designations/eu-3-26-3210